EMA orphan designation EU/3/20/2388
Active substance: human laminin-111, recombinant
Designated 2021-01-06. Orphan designation status: Positive.
First published: 2021-05-18.

Intended use: Treatment of congenital muscular dystrophy

Source: https://www.ema.europa.eu/en/medicines/human/orphan-designations/eu-3-20-2388